EMA orphan designation EU/3/14/1254
Active substance: Eculizumab
Designated 2014-03-26. Orphan designation status: Withdrawn.
First published: 2014-05-06. Last updated: 2015-03-26.

Intended use: Prevention of graft rejection following solid organ transplantation

Source: https://www.ema.europa.eu/en/medicines/human/orphan-designations/eu-3-14-1254